EMA orphan designation EU/3/16/1625
Active substance: acalabrutinib
Designated 2016-03-21. Orphan designation status: Withdrawn.
First published: 2016-05-04. Last updated: 2026-05-26.

Intended use: Treatment of mantle cell lymphoma

Source: https://www.ema.europa.eu/en/medicines/human/orphan-designations/eu-3-16-1625